EMA orphan designation EU/3/06/371
Active substance: heparin (sodium)
Designated 2006-05-22. Orphan designation status: Positive.
First published: 2009-05-15. Last updated: 2019-05-17.

Intended use: Treatment of cystic fibrosis

Source: https://www.ema.europa.eu/en/medicines/human/orphan-designations/eu-3-06-371